EMA orphan designation EU/3/26/3212
Active substance: dibotatug
Designated 2026-03-25. Orphan designation status: Positive.
First published: 2026-04-20.

Intended use: Treatment of large granular lymphocytic leukaemia

Source: https://www.ema.europa.eu/en/medicines/human/orphan-designations/eu-3-26-3212